EMA orphan designation EU/3/20/2392
Active substance: Humanised IgG1K monoclonal antibody against interferon beta
Designated 2021-01-06. Orphan designation status: Positive.
First published: 2021-05-18.

Intended use: Treatment of dermatomyositis

Source: https://www.ema.europa.eu/en/medicines/human/orphan-designations/eu-3-20-2392